EMA orphan designation EU/3/23/2832
Active substance: tasimelteon
Designated 2023-10-13. Orphan designation status: Positive.
First published: 2024-01-31.

Intended use: Treatment of Smith-Magenis syndrome

Source: https://www.ema.europa.eu/en/medicines/human/orphan-designations/eu-3-23-2832